EMA orphan designation EU/3/15/1581
Active substance: sodium phenylbutyrate
Designated 2015-11-11. Orphan designation status: Positive.
First published: 2016-01-06. Last updated: 2022-11-07.

Intended use: Treatment of pyruvate dehydrogenase complex deficiency

Source: https://www.ema.europa.eu/en/medicines/human/orphan-designations/eu-3-15-1581